EMA orphan designation EU/3/25/3096
Active substance: aglatimagene besadenovec
Designated 2025-07-18. Orphan designation status: Positive.
First published: 2025-09-04.

Intended use: Treatment of pancreatic cancer

Source: https://www.ema.europa.eu/en/medicines/human/orphan-designations/eu-3-25-3096